EMA orphan designation EU/3/03/157
Active substance: recombinant dog gastric lipase
Designated 2003-07-09. Orphan designation status: Positive.
First published: 2003-10-29. Last updated: 2003-10-29.

Intended use: Treatment of cystic fibrosis

Source: https://www.ema.europa.eu/en/medicines/human/orphan-designations/eu-3-03-157